EMA orphan designation EU/3/19/2208
Active substance: Combination of two adeno-associated viral vectors of serotype 8 containing the 5'- and the 3'- half coding sequences of human ABCA4 fused to inteins
Designated 2019-10-17. Orphan designation status: Positive.
First published: 2020-01-22. Last updated: 2022-11-07.

Intended use: Treatment of Stargardt's disease

Source: https://www.ema.europa.eu/en/medicines/human/orphan-designations/eu-3-19-2208